EMA orphan designation EU/3/13/1146
Active substance: unoprostone isopropyl
Designated 2013-06-19. Orphan designation status: Withdrawn.
First published: 2013-07-11. Last updated: 2016-08-11.

Intended use: Treatment of retinitis pigmentosa

Source: https://www.ema.europa.eu/en/medicines/human/orphan-designations/eu-3-13-1146